EMA orphan designation EU/3/22/2601
Active substance: N-(methoxypolyethylene glycol 5000 carbamoyl)-1,2-dipalmitoyl-sn-glycero-3-phosphatidylethanolamine
Designated 2022-04-13. Orphan designation status: Positive.
First published: 2022-07-19.

Intended use: Treatment in solid organ transplantation

Source: https://www.ema.europa.eu/en/medicines/human/orphan-designations/eu-3-22-2601